EMA orphan designation EU/3/24/2917
Active substance: N-(1,3-Benzothiazol-2-yl)-4-[(2-hydroxy-3-methoxyphenyl)methylamino]benzenesulfonamide
Designated 2024-05-24. Orphan designation status: Positive.
First published: 2024-08-22.

Intended use: Treatment of Platelet-activating anti-Platelet factor 4 (PF4) disorders

Source: https://www.ema.europa.eu/en/medicines/human/orphan-designations/eu-3-24-2917